EMA orphan designation EU/3/04/195
Active substance: 2-Methoxy-5-[(1Z)-2-(3,4,5-trimethoxyphenyl)ethenyl]-phenol (fosbretabulin tromethamine)
Orphan designation status: Withdrawn.
First published: 2004-11-05. Last updated: 2021-07-21.

Intended use: Treatment of anaplastic thyroid cancer

Source: https://www.ema.europa.eu/en/medicines/human/orphan-designations/eu-3-04-195